EMA orphan designation EU/3/03/142
Active substance: Iodine (131I) Anti-CEA sheep-human chimeric monoclonal antibody
Designated 2003-05-08. Orphan designation status: Withdrawn.
First published: 2003-05-21. Last updated: 2011-09-06.

Intended use: Treatment of pancreatic cancer

Source: https://www.ema.europa.eu/en/medicines/human/orphan-designations/eu-3-03-142